EMA orphan designation EU/3/24/2930
Active substance: (R)-3-(1-Cyclopropyl-3-(2-fluoro-4-(trifluoromethoxy)benzyl)ureido)piperidine-1-carboxamide
Designated 2024-06-28. Orphan designation status: Positive.
First published: 2024-08-22.

Intended use: Treatment of hyperphenylalaninaemia

Source: https://www.ema.europa.eu/en/medicines/human/orphan-designations/eu-3-24-2930